EMA orphan designation EU/3/13/1202
Active substance: tivantinib
Designated 2013-11-13. Orphan designation status: Withdrawn.
First published: 2013-12-02. Last updated: 2018-01-23.

Intended use: Treatment of hepatocellular carcinoma

Source: https://www.ema.europa.eu/en/medicines/human/orphan-designations/eu-3-13-1202